EMA orphan designation EU/3/03/147
Active substance: amiloride hydrochloride dihydrate
Designated 2003-06-30. Orphan designation status: Withdrawn.
First published: 2009-09-17. Last updated: 2009-09-17.

Intended use: Treatment of cystic fibrosis

Source: https://www.ema.europa.eu/en/medicines/human/orphan-designations/eu-3-03-147